EMA orphan designation EU/3/10/768: Enpaxiq; Epjevy
Active substance: 11-(2-pyrrolidin-1-yl-ethoxy)-14,19-dioxa-5,7,26-triaza-tetracyclo[19.3.1.1(2,6).1(8,12)] heptacosa-1(25),2(26),3,5,8,10,12(27),16,21,23-decaene
Designated 2010-08-25. Orphan designation status: Positive. EMA product number: EMEA/H/C/004193; EMEA/H/C/004793.
First published: 2010-10-18. Last updated: 2024-10-22.

Intended use: Treatment of primary myelofibrosis

Source: https://www.ema.europa.eu/en/medicines/human/orphan-designations/eu-3-10-768